EMA orphan designation EU/3/22/2656: Ordspono
Active substance: odronextamab
Designated 2022-07-18. Orphan designation status: Withdrawn. EMA product number: EMEA/H/C/006215.
First published: 2023-01-25. Last updated: 2024-06-28.

Intended use: Treatment of diffuse large B-cell lymphoma

Source: https://www.ema.europa.eu/en/medicines/human/orphan-designations/eu-3-22-2656